EMA orphan designation EU/3/22/2714
Active substance: atorvastatin
Designated 2022-11-11. Orphan designation status: Positive.
First published: 2023-01-23.

Intended use: Treatment of familial cerebral cavernous malformations

Source: https://www.ema.europa.eu/en/medicines/human/orphan-designations/eu-3-22-2714